EMA orphan designation EU/3/13/1189
Active substance: Mexiletine hydrochloride
Designated 2013-10-07. Orphan designation status: Positive.
First published: 2013-10-31. Last updated: 2013-10-31.

Intended use: Treatment of myotonic disorders

Source: https://www.ema.europa.eu/en/medicines/human/orphan-designations/eu-3-13-1189